EMA orphan designation EU/3/09/641
Active substance: alicaforsen
Designated 2009-05-15. Orphan designation status: Positive.
First published: 2009-06-09. Last updated: 2017-12-18.

Intended use: Treatment of pouchitis

Source: https://www.ema.europa.eu/en/medicines/human/orphan-designations/eu-3-09-641